EMA orphan designation EU/3/25/3130
Active substance: sonpiretigene isteparvovec
Designated 2025-08-22. Orphan designation status: Positive.
First published: 2026-04-10.

Intended use: Treatment of syndromic inherited retinal dystrophies of the cone-dominant phenotype

Source: https://www.ema.europa.eu/en/medicines/human/orphan-designations/eu-3-25-3130